EMA orphan designation EU/3/20/2309
Active substance: Bis-(3-deoxy-3-(4-(3-fluorophenyl)-1H-1,2,3-triazol-1-yl)-beta-D-galactopyranosyl) sulfane
Designated 2020-08-21. Orphan designation status: Withdrawn.
First published: 2020-11-25. Last updated: 2024-04-09.

Intended use: Treatment of idiopathic pulmonary fibrosis

Source: https://www.ema.europa.eu/en/medicines/human/orphan-designations/eu-3-20-2309